EMA orphan designation EU/3/02/113
Active substance: mitotane
Designated 2002-09-11. Orphan designation status: Withdrawn.
First published: 2005-11-29. Last updated: 2005-11-29.

Intended use: Treatment of adrenal cortical carcinoma

Source: https://www.ema.europa.eu/en/medicines/human/orphan-designations/eu-3-02-113